EMA orphan designation EU/3/02/117
Active substance: boswellia serrata
Designated 2002-10-21. Orphan designation status: Withdrawn.
First published: 2007-02-23. Last updated: 2007-02-23.

Intended use: Treatment of peritumoral oedema derived from brain tumours

Source: https://www.ema.europa.eu/en/medicines/human/orphan-designations/eu-3-02-117